EMA orphan designation EU/3/25/3055
Active substance: florbetaben (18F)
Designated 2025-05-22. Orphan designation status: Positive.
First published: 2025-06-25.

Intended use: Diagnosis of ATTR amyloidosis

Source: https://www.ema.europa.eu/en/medicines/human/orphan-designations/eu-3-25-3055